EMA orphan designation EU/3/17/1839: Omblastys
Active substance: Iodine (131I) murine IgG1 monoclonal antibody against CD276
Designated 2017-02-27. Orphan designation status: Positive. EMA product number: EMEA/H/C/005499.
First published: 2017-03-31. Last updated: 2017-03-31.

Intended use: Treatment of neuroblastoma

Source: https://www.ema.europa.eu/en/medicines/human/orphan-designations/eu-3-17-1839